EMA orphan designation EU/3/16/1643
Active substance: autologous stromal vascular cell fraction from adipose tissue
Designated 2016-04-28. Orphan designation status: Withdrawn.
First published: 2016-05-31. Last updated: 2020-08-27.

Intended use: Treatment of systemic sclerosis

Source: https://www.ema.europa.eu/en/medicines/human/orphan-designations/eu-3-16-1643